EMA orphan designation EU/3/11/933: Onivyde pegylated liposomal (previously Onivyde)
Active substance: nanoliposomal irinotecan
Designated 2011-12-09. Orphan designation status: Positive. EMA product number: EMEA/H/C/004125.
First published: 2011-12-20. Last updated: 2018-12-17.

Intended use: Treatment of pancreatic cancer

Source: https://www.ema.europa.eu/en/medicines/human/orphan-designations/eu-3-11-933